EMA orphan designation EU/3/04/222
Active substance: Pancreatic enzymes (cross linked enzyme crystal lipase, protease, amylase)
Designated 2004-09-02. Orphan designation status: Positive.
First published: 2008-08-18. Last updated: 2015-03-26.

Intended use: Treatment of malabsorption due to exocrine pancreatic enzyme insufficiency

Source: https://www.ema.europa.eu/en/medicines/human/orphan-designations/eu-3-04-222